EMA orphan designation EU/3/15/1520
Active substance: cannabidiol
Designated 2015-07-28. Orphan designation status: Positive.
First published: 2015-08-18. Last updated: 2023-06-14.

Intended use: Treatment of perinatal asphyxia

Source: https://www.ema.europa.eu/en/medicines/human/orphan-designations/eu-3-15-1520